EMA orphan designation EU/3/16/1748
Active substance: crenolanib besilate
Designated 2016-10-14. Orphan designation status: Positive.
First published: 2016-11-15. Last updated: 2016-11-15.

Intended use: Treatment of acute myeloid leukaemia

Source: https://www.ema.europa.eu/en/medicines/human/orphan-designations/eu-3-16-1748